EMA orphan designation EU/3/21/2407
Active substance: autologous CD34+ cells transduced with a lentiviral RNA vector that results in integrated cDNA encoding for functional cystinosin
Designated 2021-02-19. Orphan designation status: Positive.
First published: 2022-02-23. Last updated: 2024-04-08.

Intended use: Treatment of cystinosis

Source: https://www.ema.europa.eu/en/medicines/human/orphan-designations/eu-3-21-2407